EMA orphan designation EU/3/15/1452
Active substance: tideglusib
Designated 2015-03-19. Orphan designation status: Withdrawn.
First published: 2015-05-11. Last updated: 2019-02-21.

Intended use: Treatment of fragile X syndrome

Source: https://www.ema.europa.eu/en/medicines/human/orphan-designations/eu-3-15-1452